EMA orphan designation EU/3/06/408
Active substance: human cytomegalovirus immunoglobulin
Designated 2006-10-31. Orphan designation status: Positive.
First published: 2009-05-15. Last updated: 2009-05-15.

Intended use: Prevention of congenital cytomegalovirus infection following primary cytomegalovirus infection

Source: https://www.ema.europa.eu/en/medicines/human/orphan-designations/eu-3-06-408